EMA orphan designation EU/3/23/2781
Active substance: Humanised IgG1 kappa fragment antibody targeting TfR1 conjugated to P125 oligonucleotide
Designated 2023-05-22. Orphan designation status: Positive.
First published: 2024-02-02.

Intended use: Treatment of myotonic disorders

Source: https://www.ema.europa.eu/en/medicines/human/orphan-designations/eu-3-23-2781